EMA orphan designation EU/3/25/3106
Active substance: IgG1 trispecific monoclonal antibody against T-cell receptor CD3, B-cell maturation antigen and G protein-coupled receptor class C group 5 member D
Orphan designation status: Positive.
First published: 2026-04-10.

Intended use: Treatment of multiple myeloma

Source: https://www.ema.europa.eu/en/medicines/human/orphan-designations/eu-3-25-3106